EMA orphan designation EU/3/15/1477
Active substance: Human reovirus type 3 Dearing strain
Designated 2015-04-24. Orphan designation status: Positive.
First published: 2015-05-21. Last updated: 2015-05-21.

Intended use: Treatment of pancreatic cancer

Source: https://www.ema.europa.eu/en/medicines/human/orphan-designations/eu-3-15-1477